EMA orphan designation EU/3/18/2096
Active substance: imlifidase
Designated 2018-11-19. Orphan designation status: Positive.
First published: 2019-02-13.

Intended use: Treatment of anti-glomerular basement membrane disease

Source: https://www.ema.europa.eu/en/medicines/human/orphan-designations/eu-3-18-2096